EMA orphan designation EU/3/19/2148
Active substance: 4-hydroxy-6-{2-[4-(trifluoromethyl)phenyl]ethyl}pyridazin-3(2H)-one
Designated 2019-04-01. Orphan designation status: Withdrawn.
First published: 2019-05-07. Last updated: 2019-11-20.

Intended use: Treatment of Friedreich’s ataxia

Source: https://www.ema.europa.eu/en/medicines/human/orphan-designations/eu-3-19-2148